EMA orphan designation EU/3/17/1885
Active substance: sildenafil
Designated 2017-06-20. Orphan designation status: Positive.
First published: 2017-07-17. Last updated: 2017-07-17.

Intended use: Treatment of congenital diaphragmatic hernia

Source: https://www.ema.europa.eu/en/medicines/human/orphan-designations/eu-3-17-1885